EMA orphan designation EU/3/24/2962
Active substance: obrixtamig
Designated 2024-08-21. Orphan designation status: Positive.
First published: 2024-09-18.

Intended use: Treatment of extrapulmonary neuroendocrine carcinoma

Source: https://www.ema.europa.eu/en/medicines/human/orphan-designations/eu-3-24-2962